EMA orphan designation EU/3/15/1504
Active substance: obinutuzumab
Designated 2015-06-19. Orphan designation status: Withdrawn.
First published: 2015-07-27. Last updated: 2025-11-07.

Intended use: Treatment of follicular lymphoma

Source: https://www.ema.europa.eu/en/medicines/human/orphan-designations/eu-3-15-1504